EMA orphan designation EU/3/18/1978
Active substance: flucytosine
Designated 2018-02-22. Orphan designation status: Positive.
First published: 2018-04-10. Last updated: 2024-10-11.

Intended use: Treatment of glioma

Source: https://www.ema.europa.eu/en/medicines/human/orphan-designations/eu-3-18-1978